EMA orphan designation EU/3/07/492
Active substance: Iodine (131I) chlorotoxin
Designated 2007-10-22. Orphan designation status: Withdrawn.
First published: 2009-09-21. Last updated: 2019-02-21.

Intended use: Treatment of glioma

Source: https://www.ema.europa.eu/en/medicines/human/orphan-designations/eu-3-07-492